EMA orphan designation EU/3/05/299
Active substance: 4-Imino-1, 3-diazobicyclo-[3.1.0]-hexan-2-one
Designated 2005-07-27. Orphan designation status: Positive.
First published: 2005-11-29. Last updated: 2005-11-29.

Intended use: Treatment of pancreatic cancer

Source: https://www.ema.europa.eu/en/medicines/human/orphan-designations/eu-3-05-299